EMA orphan designation EU/3/22/2740
Active substance: N1,N14-diethyl-3S,12S-dihydroxyhomospermine tetrahydrochloride
Designated 2023-01-13. Orphan designation status: Positive.
First published: 2023-03-23.

Intended use: Treatment of pancreatic cancer

Source: https://www.ema.europa.eu/en/medicines/human/orphan-designations/eu-3-22-2740